EMA orphan designation EU/3/14/1344
Active substance: nitric oxide
Designated 2014-10-15. Orphan designation status: Positive.
First published: 2014-11-12. Last updated: 2014-11-12.

Intended use: Treatment of cystic fibrosis

Source: https://www.ema.europa.eu/en/medicines/human/orphan-designations/eu-3-14-1344